EMA orphan designation EU/3/11/888: Omjjara
Active substance: N-(cyanomethyl)-4-(2-{[4-(morpholin-4-yl)phenyl]amino}pyrimidin-4-yl)benzamide, dihydrochloride salt (momelotinib)
Designated 2011-08-05. Orphan designation status: Positive. EMA product number: EMEA/H/C/005768.
First published: 2011-08-16. Last updated: 2022-10-04.

Intended use: Treatment of primary myelofibrosis

Source: https://www.ema.europa.eu/en/medicines/human/orphan-designations/eu-3-11-888